EMA orphan designation EU/3/10/846
Active substance: paclitaxel
Designated 2011-02-23. Orphan designation status: Positive.
First published: 2011-03-04. Last updated: 2011-03-04.

Intended use: Treatment of oesophagus carcinoma

Source: https://www.ema.europa.eu/en/medicines/human/orphan-designations/eu-3-10-846